EMA orphan designation EU/3/06/425
Active substance: Complement factor H
Designated 2007-01-27. Orphan designation status: Withdrawn.
First published: 2008-07-29. Last updated: 2013-10-17.

Intended use: Atypical haemolytic uraemic syndrome

Source: https://www.ema.europa.eu/en/medicines/human/orphan-designations/eu-3-06-425